EMA orphan designation EU/3/17/1902
Active substance: N-{2-[(6-{[(2,6-dichloro-3,5-dimethoxyphenyl)carbamoyl](methyl)amino}pyrimidin-4-yl)amino]-5-(4-ethylpiperazin-1-yl)phenyl}prop-2-enamide
Designated 2017-08-23. Orphan designation status: Positive.
First published: 2017-10-17. Last updated: 2020-08-11.

Intended use: Treatment of hepatocellular carcinoma

Source: https://www.ema.europa.eu/en/medicines/human/orphan-designations/eu-3-17-1902